EMA orphan designation EU/3/10/823
Active substance: Lomitapide
Designated 2010-12-17. Orphan designation status: Withdrawn.
First published: 2011-01-20. Last updated: 2026-05-18.

Intended use: Treatment of familial chylomicronaemia

Source: https://www.ema.europa.eu/en/medicines/human/orphan-designations/eu-3-10-823